EMA orphan designation EU/3/05/262
Active substance: titanium dioxide; bisoctrizole
Designated 2005-03-03. Orphan designation status: Withdrawn.
First published: 2005-07-01. Last updated: 2013-07-31.

Intended use: Treatment of ultraviolet-A- and visible-light-induced photosensitivity disorders

Source: https://www.ema.europa.eu/en/medicines/human/orphan-designations/eu-3-05-262